EMA orphan designation EU/3/05/264
Active substance: cholest-4-en-3-one, oxime
Designated 2005-03-10. Orphan designation status: Withdrawn.
First published: 2005-10-11. Last updated: 2019-04-12.

Intended use: Treatment of 5q spinal muscular atrophy

Source: https://www.ema.europa.eu/en/medicines/human/orphan-designations/eu-3-05-264